EMA orphan designation EU/3/25/3060
Active substance: Humanised IgG1 monoclonal antibody against human epidermal growth factor receptor 2
Designated 2025-05-22. Orphan designation status: Positive.
First published: 2025-06-30.

Intended use: Treatment of gastric cancer

Source: https://www.ema.europa.eu/en/medicines/human/orphan-designations/eu-3-25-3060